EMA orphan designation EU/3/09/624
Active substance: autologous Tumor-Derived gp96 Heat Shock Protein-Peptide Complex
Designated 2009-04-29. Orphan designation status: Positive.
First published: 2009-05-12. Last updated: 2009-05-12.

Intended use: Treatment of glioma

Source: https://www.ema.europa.eu/en/medicines/human/orphan-designations/eu-3-09-624